EMA orphan designation EU/3/23/2787
Active substance: Evorpacept
Designated 2023-06-20. Orphan designation status: Positive.
First published: 2024-02-02.

Intended use: Treatment of gastric cancer

Source: https://www.ema.europa.eu/en/medicines/human/orphan-designations/eu-3-23-2787